EMA orphan designation EU/3/23/2829
Active substance: sodium selenate
Designated 2023-10-13. Orphan designation status: Positive.
First published: 2024-02-01.

Intended use: Treatment of progressive supranuclear palsy

Source: https://www.ema.europa.eu/en/medicines/human/orphan-designations/eu-3-23-2829